EMA orphan designation EU/3/21/2498
Active substance: human IgG1 monoclonal antibody against sortilin
Designated 2021-10-15. Orphan designation status: Withdrawn.
First published: 2022-01-20. Last updated: 2025-06-10.

Intended use: Treatment of frontotemporal dementia

Source: https://www.ema.europa.eu/en/medicines/human/orphan-designations/eu-3-21-2498